EMA orphan designation EU/3/21/2506
Active substance: adeno-associated viral vector serotype 5 expressing the human Cone-Rod Homeobox gene
Designated 2021-10-15. Orphan designation status: Positive.
First published: 2022-01-21.

Intended use: Treatment of cone-rod dystrophy

Source: https://www.ema.europa.eu/en/medicines/human/orphan-designations/eu-3-21-2506